EMA orphan designation EU/3/03/185
Active substance: tacrolimus hydrate
Designated 2004-01-14. Orphan designation status: Withdrawn.
First published: 2004-04-26. Last updated: 2010-10-14.

Intended use: Treatment of vernal keratoconjunctivitis

Source: https://www.ema.europa.eu/en/medicines/human/orphan-designations/eu-3-03-185